EMA orphan designation EU/3/16/1658
Active substance: 4-[(2E)-1-oxo-3-(2,6,6-trimethyl-1-cyclohexen-1-yl)-2-propen-1-yl]-1-piperazinecarboxamide
Designated 2016-05-30. Orphan designation status: Withdrawn.
First published: 2016-06-28. Last updated: 2017-08-16.

Intended use: Treatment of retinitis pigmentosa

Source: https://www.ema.europa.eu/en/medicines/human/orphan-designations/eu-3-16-1658